EMA orphan designation EU/3/11/899: Ninlaro
Active substance: 2,2'-{2-[(1R)-1-({[(2,5-dichlorobenzoyl)amino]acetyl}amino)-3-methylbutyl]-5-oxo-1,3,2-dioxaborolane-4,4-diyl}diacetic acid
Designated 2011-09-27. Orphan designation status: Positive. EMA product number: EMEA/H/C/003844.
First published: 2016-12-07. Last updated: 2020-08-26.

Intended use: Treatment of multiple myeloma

Source: https://www.ema.europa.eu/en/medicines/human/orphan-designations/eu-3-11-899